EMA orphan designation EU/3/17/1870: Kygevvi
Active substance: thymidine; deoxycytidine
Designated 2017-04-20. Orphan designation status: Positive. EMA product number: EMEA/H/C/005119.
First published: 2017-05-15. Last updated: 2023-04-25.

Intended use: Treatment of mitochondrial DNA depletion syndrome, myopathic form

Source: https://www.ema.europa.eu/en/medicines/human/orphan-designations/eu-3-17-1870